EMA orphan designation EU/3/18/2030
Active substance: 20-hydroxyecdysone
Designated 2018-06-27. Orphan designation status: Positive.
First published: 2018-08-21. Last updated: 2018-08-21.

Intended use: Treatment of Duchenne muscular dystrophy

Source: https://www.ema.europa.eu/en/medicines/human/orphan-designations/eu-3-18-2030